EMA orphan designation EU/3/13/1179
Active substance: recombinant human growth hormone modified by fusion with two hydrophilic polypeptide chains (somavaratan)
Designated 2013-08-05. Orphan designation status: Withdrawn.
First published: 2013-09-13. Last updated: 2019-02-21.

Intended use: Treatment of growth hormone deficiency

Source: https://www.ema.europa.eu/en/medicines/human/orphan-designations/eu-3-13-1179